EMA orphan designation EU/3/05/307
Active substance: Mecasermin
Designated 2005-08-26. Orphan designation status: Positive.
First published: 2007-07-04. Last updated: 2007-07-04.

Intended use: Treatment of primary growth hormone insensitivity syndrome

Source: https://www.ema.europa.eu/en/medicines/human/orphan-designations/eu-3-05-307